EMA orphan designation EU/3/18/2018
Active substance: autologous CD4+ and CD8+ T cells expressing a CD19-specific chimeric antigen receptor (lisocabtagene maraleucel)
Designated 2018-05-25. Orphan designation status: Withdrawn.
First published: 2018-07-24. Last updated: 2022-05-04.

Intended use: Treatment of follicular lymphoma

Source: https://www.ema.europa.eu/en/medicines/human/orphan-designations/eu-3-18-2018